EMA orphan designation EU/3/23/2885
Active substance: motixafortide
Designated 2024-01-12. Orphan designation status: Positive.
First published: 2024-04-11.

Intended use: Treatment of patients undergoing haematopoietic stem cell transplantation

Source: https://www.ema.europa.eu/en/medicines/human/orphan-designations/eu-3-23-2885